EMA orphan designation EU/3/10/834
Active substance: Human plasmin
Designated 2011-02-23. Orphan designation status: Withdrawn.
First published: 2011-03-04. Last updated: 2018-03-12.

Intended use: Treatment of acute peripheral arterial occlusion

Source: https://www.ema.europa.eu/en/medicines/human/orphan-designations/eu-3-10-834